EMA orphan designation EU/3/21/2432
Active substance: 2-[4-[3-(methylamino)-1-phenylpropoxy]phenyl]ethanol hydrochloride
Designated 2021-05-20. Orphan designation status: Positive.
First published: 2022-03-17.

Intended use: Treatment of fragile X syndrome

Source: https://www.ema.europa.eu/en/medicines/human/orphan-designations/eu-3-21-2432